EMA orphan designation EU/3/10/847
Active substance: pegylated B-domain-deleted sequence-modified recombinant human factor VIII (damoctocog alfa pegol)
Designated 2011-02-23. Orphan designation status: Withdrawn.
First published: 2011-03-07. Last updated: 2019-01-28.

Intended use: Treatment of haemophilia A

Source: https://www.ema.europa.eu/en/medicines/human/orphan-designations/eu-3-10-847